EMA orphan designation EU/3/09/642
Active substance: chimeric anti-interleukin-6 monoclonal antibody
Designated 2009-06-12. Orphan designation status: Withdrawn.
First published: 2009-07-01. Last updated: 2015-03-06.

Intended use: Treatment of multiple myeloma

Source: https://www.ema.europa.eu/en/medicines/human/orphan-designations/eu-3-09-642